EMA orphan designation EU/3/16/1691
Active substance: 2-[4-(1-methyl-4-pyridin-4-yl-1H-pyrazol-3-yl)-phenoxymethyl]-quinoline succinic acid
Designated 2016-07-14. Orphan designation status: Withdrawn.
First published: 2016-09-06. Last updated: 2018-05-29.

Intended use: Treatment of Huntington’s disease

Source: https://www.ema.europa.eu/en/medicines/human/orphan-designations/eu-3-16-1691